EMA orphan designation EU/3/21/2542
Active substance: atrasentan
Designated 2021-12-10. Orphan designation status: Positive.
First published: 2022-04-19. Last updated: 2024-10-22.

Intended use: Treatment of primary IgA nephropathy

Source: https://www.ema.europa.eu/en/medicines/human/orphan-designations/eu-3-21-2542